EMA orphan designation EU/3/23/2779
Active substance: 2-((2-fluoro-4-iodophenyl)amino)-N-(2-hydroxyethoxy)-1-methyl-1H-pyrrolo[2,3-b]pyridine-3-carboxamide
Designated 2023-05-22. Orphan designation status: Positive.
First published: 2024-02-02. Last updated: 2026-05-11.

Intended use: Treatment of neurofibromatosis type 1

Source: https://www.ema.europa.eu/en/medicines/human/orphan-designations/eu-3-23-2779